EMA orphan designation EU/3/13/1132
Active substance: N-methyl-4-({4-[({3-methyl(methylsulfonyl)aminopyrazin-2-yl}methyl)amino]-5-(trifluoromethyl)pyrimidin-2-yl}amino)benzamide hydrochloride (defactinib)
Designated 2013-06-07. Orphan designation status: Withdrawn.
First published: 2013-06-25. Last updated: 2022-02-28.

Intended use: Treatment of malignant mesothelioma

Source: https://www.ema.europa.eu/en/medicines/human/orphan-designations/eu-3-13-1132